EMA orphan designation EU/3/15/1596
Active substance: bilayer engineered collagen hydrogel-based skin graft composed of autologous keratinocytes and fibroblasts
Designated 2015-12-14. Orphan designation status: Positive.
First published: 2016-02-03. Last updated: 2022-08-03.

Intended use: Treatment of partial deep dermal and full-thickness burns

Source: https://www.ema.europa.eu/en/medicines/human/orphan-designations/eu-3-15-1596